EMA orphan designation EU/3/24/3000
Active substance: roginolisib
Designated 2024-12-13. Orphan designation status: Positive.
First published: 2025-01-13.

Intended use: Treatment of uveal melanoma

Source: https://www.ema.europa.eu/en/medicines/human/orphan-designations/eu-3-24-3000